EMA orphan designation EU/3/23/2846
Active substance: sodium oxybate
Designated 2023-10-13. Orphan designation status: Positive.
First published: 2024-02-01.

Intended use: Treatment of narcolepsy

Source: https://www.ema.europa.eu/en/medicines/human/orphan-designations/eu-3-23-2846